EMA orphan designation EU/3/19/2199
Active substance: veledimex
Designated 2019-08-21. Orphan designation status: Withdrawn.
First published: 2020-01-16. Last updated: 2022-03-21.

Intended use: Treatment of glioma

Source: https://www.ema.europa.eu/en/medicines/human/orphan-designations/eu-3-19-2199